EMA orphan designation EU/3/16/1622: Roctavian
Active substance: adeno-associated viral vector serotype 5 containing a B-domain deleted variant of human coagulation factor VIII gene
Designated 2016-03-21. Orphan designation status: Positive. EMA product number: EMEA/H/C/004749; EMEA/H/C/005830.
First published: 2016-05-04. Last updated: 2022-09-28.

Intended use: Treatment of haemophilia A

Source: https://www.ema.europa.eu/en/medicines/human/orphan-designations/eu-3-16-1622